EMA orphan designation EU/3/04/193
Active substance: anti-epithelial cell adhesion molecule/anti-CD3 monoclonal antibody
Designated 2004-03-08. Orphan designation status: Positive.
First published: 2005-10-27. Last updated: 2014-02-07.

Intended use: Treatment of ovarian cancer

Source: https://www.ema.europa.eu/en/medicines/human/orphan-designations/eu-3-04-193